EMA orphan designation EU/3/24/2992
Active substance: 3-chloro-4-fluorophenyl-(4-fluoro-4-(((5-methylpyrimidin-2-ylmethyl)amino)methyl)piperidin-1yl)methanone
Designated 2024-11-11. Orphan designation status: Positive.
First published: 2024-11-21.

Intended use: Treatment of fragile X syndrome

Source: https://www.ema.europa.eu/en/medicines/human/orphan-designations/eu-3-24-2992